EMA orphan designation EU/3/18/2019: Imbarkyd
Active substance: Bardoxolone methyl
Designated 2018-05-25. Orphan designation status: Positive. EMA product number: EMEA/H/C/005869.
First published: 2018-07-23. Last updated: 2023-01-30.

Intended use: Treatment of Alport syndrome

Source: https://www.ema.europa.eu/en/medicines/human/orphan-designations/eu-3-18-2019